EMA orphan designation EU/3/14/1293
Active substance: recombinant monoclonal antibody to human serum amyloid P component (dezamizumab)
Designated 2014-07-29. Orphan designation status: Withdrawn.
First published: 2014-09-01. Last updated: 2019-07-09.

Intended use: Treatment of AL amyloidosis

Source: https://www.ema.europa.eu/en/medicines/human/orphan-designations/eu-3-14-1293